EMA orphan designation EU/3/19/2142
Active substance: losartan
Designated 2019-02-26. Orphan designation status: Positive.
First published: 2019-04-09. Last updated: 2022-03-07.

Intended use: Treatment of epidermolysis bullosa

Source: https://www.ema.europa.eu/en/medicines/human/orphan-designations/eu-3-19-2142